EMA orphan designation EU/3/02/110
Active substance: thymalfasin
Designated 2002-07-30. Orphan designation status: Positive.
First published: 2003-01-08. Last updated: 2003-01-08.

Intended use: Treatment of hepatocellular carcinoma

Source: https://www.ema.europa.eu/en/medicines/human/orphan-designations/eu-3-02-110